EMA orphan designation EU/3/07/523: Lutathera
Active substance: Lutetium (177Lu)-N-[(4,7,10-Tricarboxymethyl-1,4,7,10-tetraazacyclododec-1-yl)acetyl]-D-phenylalanyl-L-cysteinyl-L-tyrosyl-D-tryptophanyl-L-lysyl-L-threoninyl-L-cysteinyl-L-threonine-cyclic(2-7)disulfide (lutetium (177Lu) oxodotreotide)
Designated 2008-01-31. Orphan designation status: Positive. EMA product number: EMEA/H/C/004123.
First published: 2008-07-10. Last updated: 2023-05-03.

Intended use: Treatment of gastro-entero-pancreatic neuroendocrine tumours

Source: https://www.ema.europa.eu/en/medicines/human/orphan-designations/eu-3-07-523